EMA orphan designation EU/3/15/1601
Active substance: sodium benzoate
Designated 2016-01-11. Orphan designation status: Positive.
First published: 2016-02-05. Last updated: 2019-08-19.

Intended use: Treatment of argininosuccinic aciduria

Source: https://www.ema.europa.eu/en/medicines/human/orphan-designations/eu-3-15-1601